EMA orphan designation EU/3/16/1724
Active substance: nintedanib
Designated 2016-08-29. Orphan designation status: Withdrawn.
First published: 2016-10-03. Last updated: 2016-10-03.

Intended use: Treatment of systemic sclerosis

Source: https://www.ema.europa.eu/en/medicines/human/orphan-designations/eu-3-16-1724